EMA orphan designation EU/3/24/2994
Active substance: Felzartamab
Designated 2024-11-11. Orphan designation status: Positive.
First published: 2024-11-21. Last updated: 2025-07-17.

Intended use: Treatment of primary IgA nephropathy

Source: https://www.ema.europa.eu/en/medicines/human/orphan-designations/eu-3-24-2994